EMA orphan designation EU/3/14/1412
Active substance: Herpes simplex type 1 virus containing cellular B-myb gene as tumour-specific promoter
Designated 2015-01-15. Orphan designation status: Positive.
First published: 2015-03-05. Last updated: 2015-03-05.

Intended use: Treatment of pancreatic cancer

Source: https://www.ema.europa.eu/en/medicines/human/orphan-designations/eu-3-14-1412